EMA orphan designation EU/3/24/2894
Active substance: mRNA encoding the human CFTR gene
Designated 2024-02-19. Orphan designation status: Positive.
First published: 2024-04-11.

Intended use: Treatment of cystic fibrosis

Source: https://www.ema.europa.eu/en/medicines/human/orphan-designations/eu-3-24-2894